EMA orphan designation EU/3/24/2923
Active substance: 7-ethyl-10-hydroxy-camptothecin; irinotecan hydrochloride trihydrate
Designated 2024-05-24. Orphan designation status: Positive.
First published: 2024-08-22.

Intended use: Treatment of small cell lung cancer

Source: https://www.ema.europa.eu/en/medicines/human/orphan-designations/eu-3-24-2923